EMA orphan designation EU/3/05/318
Active substance: recombinant modified vaccinia virus Ankara expressing tuberculosis antigen 85A
Designated 2005-10-28. Orphan designation status: Withdrawn.
First published: 2009-09-23. Last updated: 2016-01-19.

Intended use: Prevention of tuberculosis disease in Bacille-Calmette-Guerin-vaccinated individuals

Source: https://www.ema.europa.eu/en/medicines/human/orphan-designations/eu-3-05-318